EMA orphan designation EU/3/17/1837
Active substance: Humanised IgG4 monoclonal antibody to the human toll-like receptor type 2
Designated 2017-02-27. Orphan designation status: Positive.
First published: 2017-03-31. Last updated: 2017-03-31.

Intended use: Treatment of myelodysplastic syndromes

Source: https://www.ema.europa.eu/en/medicines/human/orphan-designations/eu-3-17-1837